EMA orphan designation EU/3/15/1599
Active substance: Adeno-associated viral vector serotype rh10 containing the human factor IX gene for the treatment of haemophilia B-
Designated 2015-12-14. Orphan designation status: Withdrawn.
First published: 2016-02-03. Last updated: 2022-10-11.

Intended use: Treatment of haemophilia B

Source: https://www.ema.europa.eu/en/medicines/human/orphan-designations/eu-3-15-1599